EMA orphan designation EU/3/24/2998
Active substance: vosoritide
Designated 2024-12-13. Orphan designation status: Positive.
First published: 2025-01-13.

Intended use: Treatment of hypochondroplasia

Source: https://www.ema.europa.eu/en/medicines/human/orphan-designations/eu-3-24-2998